EMA orphan designation EU/3/17/1951
Active substance: agammaglobulinaemia tyrosine kinase
Designated 2017-12-12. Orphan designation status: Withdrawn.
First published: 2018-01-24. Last updated: 2021-11-15.

Intended use: Treatment of pemphigus

Source: https://www.ema.europa.eu/en/medicines/human/orphan-designations/eu-3-17-1951